EMA orphan designation EU/3/24/2890
Active substance: raludotatug deruxtecan
Designated 2024-02-19. Orphan designation status: Positive.
First published: 2024-04-11.

Intended use: Treatment of ovarian cancer

Source: https://www.ema.europa.eu/en/medicines/human/orphan-designations/eu-3-24-2890